EMA orphan designation EU/3/10/753
Active substance: 6alpha-ethyl-chenodeoxycholic acid
Designated 2010-07-27. Orphan designation status: Withdrawn.
First published: 2010-08-05. Last updated: 2022-11-21.

Intended use: Treatment of primary biliary cirrhosis

Source: https://www.ema.europa.eu/en/medicines/human/orphan-designations/eu-3-10-753